EMA orphan designation EU/3/10/781
Active substance: glutathione-pegylated liposomal doxorubicin hydrochloride
Designated 2010-09-20. Orphan designation status: Withdrawn.
First published: 2010-10-18. Last updated: 2015-05-08.

Intended use: Treatment of glioma

Source: https://www.ema.europa.eu/en/medicines/human/orphan-designations/eu-3-10-781